EMA orphan designation EU/3/24/2950
Active substance: rivoceranib (mesilate)
Designated 2024-07-25. Orphan designation status: Positive.
First published: 2024-09-13. Last updated: 2024-10-31.

Intended use: Treatment of hepatocellular carcinoma

Source: https://www.ema.europa.eu/en/medicines/human/orphan-designations/eu-3-24-2950